EMA orphan designation EU/3/08/563
Active substance: recombinant derivative of C3 transferase
Designated 2008-09-05. Orphan designation status: Positive.
First published: 2009-04-24. Last updated: 2022-01-25.

Intended use: Treatment of traumatic spinal cord injury

Source: https://www.ema.europa.eu/en/medicines/human/orphan-designations/eu-3-08-563